EMA orphan designation EU/3/14/1303
Active substance: cediranib
Designated 2014-07-29. Orphan designation status: Positive.
First published: 2014-09-01. Last updated: 2014-09-01.

Intended use: Treatment of ovarian cancer

Source: https://www.ema.europa.eu/en/medicines/human/orphan-designations/eu-3-14-1303